EMA orphan designation EU/3/19/2198
Active substance: adenoviral vector serotype 5 encoding the human interleukin-12 p70 transgene under the control of activator ligand veledimex
Designated 2019-08-21. Orphan designation status: Withdrawn.
First published: 2020-01-16. Last updated: 2022-03-21.

Intended use: Treatment of glioma

Source: https://www.ema.europa.eu/en/medicines/human/orphan-designations/eu-3-19-2198